EMA orphan designation EU/3/09/699
Active substance: recombinant chimeric monoclonal antibody against CD20 (ublituximab)
Designated 2009-11-26. Orphan designation status: Positive.
First published: 2009-12-09. Last updated: 2022-11-22.

Intended use: Treatment of chronic lymphocytic leukaemia

Source: https://www.ema.europa.eu/en/medicines/human/orphan-designations/eu-3-09-699